EMA orphan designation EU/3/19/2229
Active substance: adeno-associated virus vector encoding human phenylalanine hydroxylase
Designated 2019-12-16. Orphan designation status: Withdrawn.
First published: 2020-02-25.

Intended use: Treatment of phenylalanine hydroxylase deficiency

Source: https://www.ema.europa.eu/en/medicines/human/orphan-designations/eu-3-19-2229